EMA orphan designation EU/3/25/3154
Active substance: antisense oligonucleotide against ABCA4 pre-mRNA
Designated 2025-11-21. Orphan designation status: Positive.
First published: 2026-04-10.

Intended use: Treatment of non-syndromic inherited retinal dystrophies due to defects in the ABCA4 gene

Source: https://www.ema.europa.eu/en/medicines/human/orphan-designations/eu-3-25-3154